EMA orphan designation EU/3/19/2154
Active substance: balipodect
Designated 2019-04-14. Orphan designation status: Positive.
First published: 2019-06-14. Last updated: 2020-08-26.

Intended use: Treatment of fragile X syndrome

Source: https://www.ema.europa.eu/en/medicines/human/orphan-designations/eu-3-19-2154